EMA orphan designation EU/3/09/666
Active substance: Eicosapentaenoic acid
Designated 2009-10-08. Orphan designation status: Positive.
First published: 2009-10-20. Last updated: 2021-04-22.

Intended use: Treatment of familial adenomatous polyposis (FAP)

Source: https://www.ema.europa.eu/en/medicines/human/orphan-designations/eu-3-09-666